EMA orphan designation EU/3/08/597
Active substance: 2,3,4,5 Tetrahydro-2,8-dimethyl-5-[2-(6-methyl-3-pyridinyl)ethyl]-1H-pyrido[4,3-b]indole dihydrochloride
Designated 2009-01-21. Orphan designation status: Withdrawn.
First published: 2009-06-15. Last updated: 2015-05-08.

Intended use: Treatment of Huntington’s disease

Source: https://www.ema.europa.eu/en/medicines/human/orphan-designations/eu-3-08-597